EMA orphan designation EU/3/21/2417
Active substance: adeno-associated virus serotype hu68 containing the human GALC gene
Designated 2021-03-26. Orphan designation status: Positive.
First published: 2022-01-19.

Intended use: Treatment of Krabbe disease

Source: https://www.ema.europa.eu/en/medicines/human/orphan-designations/eu-3-21-2417